EMA orphan designation EU/3/04/258
Active substance: L-Asparaginase
Designated 2005-01-26. Orphan designation status: Withdrawn.
First published: 2005-07-26. Last updated: 2018-02-12.

Intended use: Treatment of acute lymphoblastic leukaemia

Source: https://www.ema.europa.eu/en/medicines/human/orphan-designations/eu-3-04-258